EMA orphan designation EU/3/15/1570
Active substance: azacitidine
Designated 2015-11-11. Orphan designation status: Withdrawn.
First published: 2016-01-06. Last updated: 2017-02-08.

Intended use: Treatment of nasopharyngeal carcinoma

Source: https://www.ema.europa.eu/en/medicines/human/orphan-designations/eu-3-15-1570